EMA orphan designation EU/3/13/1137
Active substance: 4,6,4'-trimethylangelicin
Designated 2013-06-19. Orphan designation status: Positive.
First published: 2013-07-11. Last updated: 2020-06-08.

Intended use: Treatment of cystic fibrosis

Source: https://www.ema.europa.eu/en/medicines/human/orphan-designations/eu-3-13-1137